EMA orphan designation EU/3/22/2666
Active substance: W253R/R275S tissue plasminogen activator
Designated 2022-07-18. Orphan designation status: Positive.
First published: 2023-01-23.

Intended use: Treatment of non-traumatic spontaneous intracerebral haemorrhage

Source: https://www.ema.europa.eu/en/medicines/human/orphan-designations/eu-3-22-2666